EMA orphan designation EU/3/20/2379
Active substance: synthetic oligonucleotide selectively targeting UBE3A antisense RNA transcripts
Designated 2020-12-09. Orphan designation status: Positive.
First published: 2021-05-27. Last updated: 2025-06-10.

Intended use: Treatment of Angelman syndrome

Source: https://www.ema.europa.eu/en/medicines/human/orphan-designations/eu-3-20-2379